EMA orphan designation EU/3/05/341
Active substance: Imexon
Designated 2005-12-23. Orphan designation status: Positive.
First published: 2006-08-08. Last updated: 2018-10-04.

Intended use: Treatment of ovarian cancer

Source: https://www.ema.europa.eu/en/medicines/human/orphan-designations/eu-3-05-341